EMA orphan designation EU/3/10/769: Enpaxiq; Epjevy
Active substance: 11-(2-pyrrolidin-1-yl-ethoxy)-14,19-dioxa-5,7,26-triaza-tetracyclo[19.3.1.1(2,6).1(8,12)] heptacosa-1(25),2(26),3,5,8,10,12(27),16,21,23-decaene
Designated 2010-08-25. Orphan designation status: Positive. EMA product number: EMEA/H/C/004193; EMEA/H/C/004793.
First published: 2010-10-18. Last updated: 2024-10-22.

Intended use: Treatment of post-polycythaemia vera myelofibrosis

Source: https://www.ema.europa.eu/en/medicines/human/orphan-designations/eu-3-10-769